EMA orphan designation EU/3/03/178
Active substance: sildenafil citrate
Designated 2003-12-12. Orphan designation status: Expired.
First published: 2007-02-28. Last updated: 2016-01-19.

Intended use: Treatment of pulmonary arterial hypertension and chronic thromboembolic pulmonary hypertension

Source: https://www.ema.europa.eu/en/medicines/human/orphan-designations/eu-3-03-178